EMA orphan designation EU/3/00/005: Mylotarg
Active substance: gemtuzumab ozogamicin
Designated 2000-10-18. Orphan designation status: Positive. EMA product number: EMEA/H/C/004204; EMEA/H/C/000705.
First published: 2004-11-08. Last updated: 2013-06-19.

Intended use: Treatment of acute myeloid leukaemia

Source: https://www.ema.europa.eu/en/medicines/human/orphan-designations/eu-3-00-005